EMA orphan designation EU/3/21/2421
Active substance: Herpes simplex virus 1 expressing the human CFTR gene
Designated 2021-03-26. Orphan designation status: Positive.
First published: 2022-01-19. Last updated: 2023-01-17.

Intended use: Treatment of cystic fibrosis

Source: https://www.ema.europa.eu/en/medicines/human/orphan-designations/eu-3-21-2421